EMA orphan designation EU/3/17/1909
Active substance: Salmonella typhi Ty21a strain transfected with a plasmid vector encoding the human vascular endothelial growth factor receptor 2
Designated 2017-08-23. Orphan designation status: Positive.
First published: 2017-10-17. Last updated: 2021-03-22.

Intended use: Treatment of glioma

Source: https://www.ema.europa.eu/en/medicines/human/orphan-designations/eu-3-17-1909